EMA orphan designation EU/3/03/149
Active substance: Cytochrome P450 isoform 2B1 gene transfected human embryonic kidney 293 cells encapsulated in polymeric cellulose sulfate
Designated 2003-06-30. Orphan designation status: Positive.
First published: 2009-09-21. Last updated: 2015-11-26.

Intended use: Treatment of pancreatic cancer in combination with ifosfamide

Source: https://www.ema.europa.eu/en/medicines/human/orphan-designations/eu-3-03-149